EMA orphan designation EU/3/15/1507
Active substance: 3-{[2,3,5,6-tetrafluoro-3'-(trifluoromethoxy)biphenyl-4-yl]carbamoyl}thiophene-2-carboxylic acid
Designated 2015-06-19. Orphan designation status: Positive.
First published: 2015-07-27. Last updated: 2023-09-18.

Intended use: Treatment of non-infectious uveitis

Source: https://www.ema.europa.eu/en/medicines/human/orphan-designations/eu-3-15-1507